EMA orphan designation EU/3/02/090
Active substance: Human transferrin conjugated to mutant diptheria toxin
Designated 2002-03-19. Orphan designation status: Withdrawn.
First published: 2007-12-04. Last updated: 2007-12-04.

Intended use: Treatment of glioma

Source: https://www.ema.europa.eu/en/medicines/human/orphan-designations/eu-3-02-090